EMA orphan designation EU/3/22/2736
Active substance: adeno-associated virus serotype rh79 containing the human OTC gene, adeno-associated virus serotype rh79 encoding a meganuclease for targeted editing of the human PCSK9 gene
Designated 2022-12-09. Orphan designation status: Positive.
First published: 2023-03-13. Last updated: 2025-06-10.

Intended use: Treatment of ornithine transcarbamylase deficiency

Source: https://www.ema.europa.eu/en/medicines/human/orphan-designations/eu-3-22-2736